EMA orphan designation EU/3/13/1178
Active substance: pegylated recombinant anti-Pseudomonas aeruginosa antibody
Designated 2013-08-05. Orphan designation status: Withdrawn.
First published: 2013-09-13. Last updated: 2015-03-06.

Intended use: Treatment of Pseudomonas aeruginosa lung infection in cystic fibrosis

Source: https://www.ema.europa.eu/en/medicines/human/orphan-designations/eu-3-13-1178